EMA orphan designation EU/3/13/1184
Designated 2013-08-05. Orphan designation status: Withdrawn.
First published: 2013-09-16. Last updated: 2026-05-13.

Intended use: Treatment of sickle cell disease

Source: https://www.ema.europa.eu/en/medicines/human/orphan-designations/eu-3-13-1184